EMA orphan designation EU/3/11/914
Active substance: smilagenin
Designated 2011-09-27. Orphan designation status: Positive.
First published: 2011-10-17. Last updated: 2019-02-22.

Intended use: Treatment of amyotrophic lateral sclerosis

Source: https://www.ema.europa.eu/en/medicines/human/orphan-designations/eu-3-11-914